EMA orphan designation EU/3/18/2054
Active substance: tetracosactide
Designated 2018-07-31. Orphan designation status: Positive.
First published: 2018-10-11.

Intended use: Treatment of Duchenne muscular dystrophy

Source: https://www.ema.europa.eu/en/medicines/human/orphan-designations/eu-3-18-2054